EMA orphan designation EU/3/15/1447
Active substance: olaratumab
Designated 2015-02-12. Orphan designation status: Withdrawn.
First published: 2015-04-09. Last updated: 2020-01-15.

Intended use: Treatment of soft tissue sarcoma

Source: https://www.ema.europa.eu/en/medicines/human/orphan-designations/eu-3-15-1447